EMA orphan designation EU/3/20/2270: Pyrukynd
Active substance: mitapivat sulfate
Designated 2020-04-22. Orphan designation status: Positive. EMA product number: EMEA/H/C/005540.
First published: 2020-08-12. Last updated: 2022-12-07.

Intended use: Treatment of pyruvate kinase deficiency

Source: https://www.ema.europa.eu/en/medicines/human/orphan-designations/eu-3-20-2270